EMA orphan designation EU/3/13/1204
Active substance: sirolimus
Designated 2013-11-13. Orphan designation status: Positive.
First published: 2013-12-02. Last updated: 2023-06-09.

Intended use: Prevention of arteriovenous access dysfunction in haemodialysis patients

Source: https://www.ema.europa.eu/en/medicines/human/orphan-designations/eu-3-13-1204